EMA orphan designation EU/3/07/480
Active substance: naptumomab estafenatox
Designated 2007-09-14. Orphan designation status: Positive.
First published: 2008-01-22. Last updated: 2011-11-11.

Intended use: Treatment of renal-cell carcinoma

Source: https://www.ema.europa.eu/en/medicines/human/orphan-designations/eu-3-07-480